EMA orphan designation EU/3/17/1939
Active substance: C1-esterase-inhibitor human
Designated 2017-10-12. Orphan designation status: Positive.
First published: 2018-01-12. Last updated: 2018-03-28.

Intended use: Treatment in solid organ transplantation

Source: https://www.ema.europa.eu/en/medicines/human/orphan-designations/eu-3-17-1939